EMA orphan designation EU/3/18/2028
Active substance: R)-1-(3-(aminomethyl) phenyl)-N-(5-((3-cyanophenyl)(cyclopropylmethylamino)methyl)-2-fluorophenyl)-3-(trifluoromethyl)-1H-pyrazole-5-carboxamide dihydrochloride (berotralstat)
Designated 2018-06-27. Orphan designation status: Positive.
First published: 2018-08-21. Last updated: 2021-06-01.

Intended use: Treatment of hereditary angioedema

Source: https://www.ema.europa.eu/en/medicines/human/orphan-designations/eu-3-18-2028